EMA orphan designation EU/3/23/2881
Active substance: ziftomenib
Designated 2024-01-12. Orphan designation status: Positive.
First published: 2024-04-11.

Intended use: Treatment of acute myeloid leukaemia

Source: https://www.ema.europa.eu/en/medicines/human/orphan-designations/eu-3-23-2881